EMA orphan designation EU/3/02/130
Active substance: G17(9) gastrin-Diphtheria Toxoid Conjugate
Designated 2003-01-28. Orphan designation status: Positive.
First published: 2009-09-21. Last updated: 2009-09-21.

Intended use: Treatment of gastric cancer

Source: https://www.ema.europa.eu/en/medicines/human/orphan-designations/eu-3-02-130